EMA orphan designation EU/3/05/313: Strimvelis
Active substance: autologous CD34+ cells transfected with retroviral vector containing adenosine deaminase gene
Designated 2005-08-26. Orphan designation status: Positive. EMA product number: EMEA/H/C/003854.
First published: 2006-01-04. Last updated: 2023-06-27.

Intended use: Treatment of severe combined immunodeficiency (SCID) due to adenosine deaminase (ADA) deficiency

Source: https://www.ema.europa.eu/en/medicines/human/orphan-designations/eu-3-05-313